EMA orphan designation EU/3/24/3008
Active substance: diazoxide choline
Designated 2024-12-13. Orphan designation status: Positive.
First published: 2025-01-13.

Intended use: Treatment of glycogen storage disease type I

Source: https://www.ema.europa.eu/en/medicines/human/orphan-designations/eu-3-24-3008